EMA orphan designation EU/3/25/3181
Active substance: autologous human T cells transduced with a lentiviral vector encoding a chimeric antigen receptor against the ERBB family
Designated 2026-01-09. Orphan designation status: Positive.
First published: 2026-04-10.

Intended use: Treatment of Fanconi anaemia

Source: https://www.ema.europa.eu/en/medicines/human/orphan-designations/eu-3-25-3181